EMA orphan designation EU/3/20/2255
Active substance: Luspatercept
Designated 2020-02-28. Orphan designation status: Positive.
First published: 2020-05-06. Last updated: 2021-02-18.

Intended use: Treatment of myelofibrosis

Source: https://www.ema.europa.eu/en/medicines/human/orphan-designations/eu-3-20-2255